EMA orphan designation EU/3/21/2522
Active substance: cannabidiol; Dronabinol
Designated 2021-11-12. Orphan designation status: Positive.
First published: 2022-06-08.

Intended use: Treatment of complex regional pain syndrome

Source: https://www.ema.europa.eu/en/medicines/human/orphan-designations/eu-3-21-2522